EMA orphan designation EU/3/25/3057
Active substance: Equine polyclonal immunoglobulin F(ab')2 fragments against ricin
Designated 2025-05-22. Orphan designation status: Withdrawn.
First published: 2025-06-24.

Intended use: Treatment of ricin poisoning

Source: https://www.ema.europa.eu/en/medicines/human/orphan-designations/eu-3-25-3057